EMA orphan designation EU/3/21/2545
Active substance: N-[1-((5-cyanopyridin-2-yl)methyl)-1H-pyrazol- 3-yl]-2-[4-(1-(trifluoromethyl)cyclopropyl) phenyl]acetamide
Designated 2021-12-10. Orphan designation status: Positive.
First published: 2022-04-21.

Intended use: Treatment of epileptic encephalopathy with continuous spike-and-wave during sleep

Source: https://www.ema.europa.eu/en/medicines/human/orphan-designations/eu-3-21-2545